EMA orphan designation EU/3/15/1600
Active substance: sodium benzoate
Designated 2016-01-11. Orphan designation status: Positive.
First published: 2016-02-05. Last updated: 2019-08-19.

Intended use: Treatment of hyperargininaemia

Source: https://www.ema.europa.eu/en/medicines/human/orphan-designations/eu-3-15-1600